EMA orphan designation EU/3/26/3220
Active substance: upadacitinib
Designated 2026-04-20. Orphan designation status: Positive.
First published: 2026-04-22.

Intended use: Treatment of systemic sclerosis

Source: https://www.ema.europa.eu/en/medicines/human/orphan-designations/eu-3-26-3220